EMA orphan designation EU/3/25/3061
Active substance: radiprodil
Designated 2025-05-22. Orphan designation status: Positive.
First published: 2025-06-25.

Intended use: Treatment of GRIN-related neurodevelopmental disorder

Source: https://www.ema.europa.eu/en/medicines/human/orphan-designations/eu-3-25-3061